EMA orphan designation EU/3/20/2319
Active substance: sodium (4-{(E)-3-(4-fluorophenyl)-3-[4-(3-morpholin-4-yl-prop1ynyl)phenyl]allyloxy}-2-methylphenoxy)acetate
Designated 2020-08-21. Orphan designation status: Withdrawn.
First published: 2020-11-25. Last updated: 2024-04-11.

Intended use: Treatment of long-chain 3-hydroxyacyl-coenzyme A dehydrogenase deficiency

Source: https://www.ema.europa.eu/en/medicines/human/orphan-designations/eu-3-20-2319